EMA orphan designation EU/3/01/042
Active substance: human engineered monoclonal antibody specific for transforming growth factor β2
Designated 2001-05-30. Orphan designation status: Withdrawn.
First published: 2009-09-17. Last updated: 2011-05-05.

Intended use: Prevention of scarring in glaucoma filtration surgical procedures

Source: https://www.ema.europa.eu/en/medicines/human/orphan-designations/eu-3-01-042